EMA orphan designation EU/3/22/2630
Active substance: Pirfenidone
Designated 2022-06-24. Orphan designation status: Positive.
First published: 2022-09-15.

Intended use: Treatment of idiopathic pulmonary fibrosis

Source: https://www.ema.europa.eu/en/medicines/human/orphan-designations/eu-3-22-2630